EMA orphan designation EU/3/14/1423
Active substance: synthetic signal peptide of human mucin-1 (amino acids 1-21)
Designated 2015-01-15. Orphan designation status: Positive.
First published: 2015-03-06. Last updated: 2022-06-22.

Intended use: Treatment of plasma cell myeloma

Source: https://www.ema.europa.eu/en/medicines/human/orphan-designations/eu-3-14-1423